EMA orphan designation EU/3/21/2404
Designated 2021-02-19. Orphan designation status: Positive.
First published: 2022-02-22.

Intended use: Treatment of leishmaniasis

Source: https://www.ema.europa.eu/en/medicines/human/orphan-designations/eu-3-21-2404